EMA orphan designation EU/3/08/541
Active substance: [Nle4, D-Phe7]-alfa-melanocyte stimulating hormone (afamelanotide)
Designated 2008-05-08. Orphan designation status: Expired.
First published: 2009-09-17. Last updated: 2025-01-09.

Intended use: Treatment of erythropoietic protoporphyria

Source: https://www.ema.europa.eu/en/medicines/human/orphan-designations/eu-3-08-541